EMA orphan designation EU/3/08/587
Active substance: cenersen
Designated 2008-12-03. Orphan designation status: Positive.
First published: 2009-06-29. Last updated: 2015-03-23.

Intended use: Treatment of chronic lymphocytic leukaemia

Source: https://www.ema.europa.eu/en/medicines/human/orphan-designations/eu-3-08-587